EMA orphan designation EU/3/20/2300
Active substance: fasudil hydrochloride
Designated 2020-07-27. Orphan designation status: Positive.
First published: 2020-11-19.

Intended use: Treatment of non-traumatic subarachnoid haemorrhage

Source: https://www.ema.europa.eu/en/medicines/human/orphan-designations/eu-3-20-2300